EMA orphan designation EU/3/14/1286
Active substance: Humanised Fc engineered monoclonal antibody against CD19 (tafasitamab)
Designated 2014-07-04. Orphan designation status: Positive.
First published: 2014-07-16. Last updated: 2021-11-18.

Intended use: Treatment of chronic lymphocytic leukaemia / small lymphocytic lymphoma

Source: https://www.ema.europa.eu/en/medicines/human/orphan-designations/eu-3-14-1286